EMA orphan designation EU/3/22/2655
Active substance: berzosertib
Designated 2022-07-18. Orphan designation status: Withdrawn.
First published: 2023-01-23. Last updated: 2023-02-07.

Intended use: Treatment of small cell lung cancer

Source: https://www.ema.europa.eu/en/medicines/human/orphan-designations/eu-3-22-2655